EMA orphan designation EU/3/20/2361
Active substance: perflubron
Designated 2020-11-13. Orphan designation status: Positive.
First published: 2021-02-15. Last updated: 2021-09-16.

Intended use: Treatment of congenital pulmonary hypoplasia

Source: https://www.ema.europa.eu/en/medicines/human/orphan-designations/eu-3-20-2361